EMA orphan designation EU/3/04/209
Active substance: ciclosporin
Designated 2004-07-29. Orphan designation status: Positive.
First published: 2007-12-13. Last updated: 2019-07-10.

Intended use: Prevention of graft rejection after lung transplantation

Source: https://www.ema.europa.eu/en/medicines/human/orphan-designations/eu-3-04-209